EMA orphan designation EU/3/10/787
Active substance: (3S)-3-{4-[7-(aminocarbonyl)-2H-indazol-2-yl] phenyl} piperidine tosilate monohydrate salt
Designated 2010-10-01. Orphan designation status: Withdrawn.
First published: 2010-10-14. Last updated: 2019-02-21.

Intended use: Treatment of mantle cell lymphoma

Source: https://www.ema.europa.eu/en/medicines/human/orphan-designations/eu-3-10-787